EMA orphan designation EU/3/10/774
Active substance: allogeneic human dermal fibroblasts
Designated 2010-09-20. Orphan designation status: Positive.
First published: 2010-10-18. Last updated: 2012-02-09.

Intended use: Treatment of epidermolysis bullosa

Source: https://www.ema.europa.eu/en/medicines/human/orphan-designations/eu-3-10-774